EMA orphan designation EU/3/16/1664
Active substance: polyethylene glycol-modified human recombinant truncated cystathionine beta-synthase (pegtibatinase)
Designated 2016-05-30. Orphan designation status: Positive.
First published: 2016-06-23. Last updated: 2023-02-06.

Intended use: Treatment of homocystinuria

Source: https://www.ema.europa.eu/en/medicines/human/orphan-designations/eu-3-16-1664